EMA orphan designation EU/3/13/1124
Active substance: 2-Hydroxypropyl-β-cyclodextrin
Designated 2013-04-26. Orphan designation status: Positive.
First published: 2013-05-14. Last updated: 2024-10-22.

Intended use: Treatment of Niemann-Pick's disease, type C

Source: https://www.ema.europa.eu/en/medicines/human/orphan-designations/eu-3-13-1124